EMA orphan designation EU/3/16/1665
Active substance: recombinant adeno-associated viral vector containing the human RPGR gene
Designated 2016-05-30. Orphan designation status: Positive.
First published: 2016-06-22. Last updated: 2023-03-27.

Intended use: Treatment of retinitis pigmentosa caused by mutations in the RPGR gene

Source: https://www.ema.europa.eu/en/medicines/human/orphan-designations/eu-3-16-1665